EMA orphan designation EU/3/18/2123
Active substance: 6,8-bis(benzylthio)octanoic acid
Designated 2018-12-14. Orphan designation status: Positive.
First published: 2019-03-12.

Intended use: Treatment of acute myeloid leukaemia

Source: https://www.ema.europa.eu/en/medicines/human/orphan-designations/eu-3-18-2123